EMA orphan designation EU/3/04/240
Active substance: rufinamide
Designated 2004-10-20. Orphan designation status: Expired.
First published: 2007-02-28. Last updated: 2019-02-20.

Intended use: Treatment of Lennox-Gastaut syndrome

Source: https://www.ema.europa.eu/en/medicines/human/orphan-designations/eu-3-04-240